EMA orphan designation EU/3/08/557
Active substance: sapacitabine
Designated 2008-07-08. Orphan designation status: Positive.
First published: 2009-05-15. Last updated: 2009-05-15.

Intended use: Treatment of myelodysplastic syndromes

Source: https://www.ema.europa.eu/en/medicines/human/orphan-designations/eu-3-08-557